EMA orphan designation EU/3/10/730
Active substance: raloxifene hydrochloride
Designated 2010-06-10. Orphan designation status: Positive.
First published: 2010-06-24. Last updated: 2010-06-24.

Intended use: Treatment of hereditary haemorrhagic telangiectasia

Source: https://www.ema.europa.eu/en/medicines/human/orphan-designations/eu-3-10-730